EMA orphan designation EU/3/12/960
Active substance: Glucagon
Designated 2012-03-05. Orphan designation status: Positive.
First published: 2012-04-19. Last updated: 2012-04-19.

Intended use: Treatment of congenital hyperinsulinism

Source: https://www.ema.europa.eu/en/medicines/human/orphan-designations/eu-3-12-960